EMA orphan designation EU/3/14/1317
Active substance: (Z)-3-(3-(3,5-bis(trifluoromethyl)phenyl)-1H-1,2,4-triazol-1-yl)-N'-(pyrazin-2-yl)acrylohydrazide
Designated 2014-08-22. Orphan designation status: Positive.
First published: 2014-10-01. Last updated: 2026-05-11.

Intended use: Treatment of diffuse large B-cell lymphoma

Source: https://www.ema.europa.eu/en/medicines/human/orphan-designations/eu-3-14-1317